EMA orphan designation EU/3/25/3155
Active substance: zanidatamab
Designated 2025-11-21. Orphan designation status: Positive.
First published: 2026-04-10.

Intended use: Treatment of oesophageal cancer

Source: https://www.ema.europa.eu/en/medicines/human/orphan-designations/eu-3-25-3155